EMA orphan designation EU/3/06/403
Active substance: 5-(2,6-Difluoro-phenoxy)-3(R,S)-{2(S)-[2(S)-(3-methoxycarbonyl-2(S)-{3-methyl-2(S)-[(quinoline-2-carbonyl)-amino]-butyrylamino}-propionylamino)-3-methyl-butyrylamino]-propionylamino}-4-oxo-pentanoic acid methyl ester
Designated 2006-10-23. Orphan designation status: Withdrawn.
First published: 2009-10-30. Last updated: 2015-05-08.

Intended use: Treatment of neonatal brain injury

Source: https://www.ema.europa.eu/en/medicines/human/orphan-designations/eu-3-06-403